EMA orphan designation EU/3/23/2789
Active substance: fasudil hydrochloride
Designated 2023-06-20. Orphan designation status: Positive.
First published: 2024-02-02.

Intended use: Treatment of amyotrophic lateral sclerosis

Source: https://www.ema.europa.eu/en/medicines/human/orphan-designations/eu-3-23-2789